EMA orphan designation EU/3/17/1868
Active substance: N-[(1R)-1-phenylethyl]-6-{1H-pyrazolo[3,4-d]pyrimidin-4-yl}quinazolin-2-amine
Designated 2017-04-20. Orphan designation status: Withdrawn.
First published: 2017-05-15. Last updated: 2024-11-19.

Intended use: Treatment of fragile X syndrome

Source: https://www.ema.europa.eu/en/medicines/human/orphan-designations/eu-3-17-1868